EMA orphan designation EU/3/25/3093
Active substance: octreotide hydrochloride
Designated 2025-07-18. Orphan designation status: Positive.
First published: 2025-09-04.

Intended use: Treatment of autosomal dominant polycystic kidney disease

Source: https://www.ema.europa.eu/en/medicines/human/orphan-designations/eu-3-25-3093